EMA orphan designation EU/3/16/1760
Active substance: radio-iodinated (131I) anti-CD45 murine monoclonal antibody
Designated 2016-10-14. Orphan designation status: Positive.
First published: 2016-11-17. Last updated: 2022-09-14.

Intended use: Treatment in haematopoietic stem cell transplantation

Source: https://www.ema.europa.eu/en/medicines/human/orphan-designations/eu-3-16-1760